EMA orphan designation EU/3/16/1609: Enjaymo
Active substance: sutimlimab
Orphan designation status: Positive. EMA product number: EMEA/H/C/005776.
First published: 2016-04-06. Last updated: 2025-03-12.

Intended use: Treatment of autoimmune haemolytic anaemia

Source: https://www.ema.europa.eu/en/medicines/human/orphan-designations/eu-3-16-1609